EMA orphan designation EU/3/12/966
Active substance: (1-methyl-2-nitro-1H-imidazole-5-yl)methyl N,N'-bis(2-bromoethyl)diamidophosphate
Designated 2012-03-05. Orphan designation status: Withdrawn.
First published: 2012-04-19. Last updated: 2016-10-21.

Intended use: Treatment of soft tissue sarcoma

Source: https://www.ema.europa.eu/en/medicines/human/orphan-designations/eu-3-12-966